EMA orphan designation EU/3/11/871
Active substance: salirasib
Designated 2011-06-21. Orphan designation status: Withdrawn.
First published: 2011-07-12. Last updated: 2017-10-19.

Intended use: Treatment of pancreatic cancer

Source: https://www.ema.europa.eu/en/medicines/human/orphan-designations/eu-3-11-871